EMA orphan designation EU/3/24/3020
Active substance: adeno-associated virus serotype 9 containing the human RPE65 gene
Designated 2025-01-16. Orphan designation status: Positive.
First published: 2025-02-24.

Intended use: inherited retinal dystrophy due to defects in the RPE65 gene

Source: https://www.ema.europa.eu/en/medicines/human/orphan-designations/eu-3-24-3020